EMA orphan designation EU/3/19/2222
Active substance: chimeric fibril-reactive IgG1k monoclonal antibody 11-1F4
Designated 2019-11-13. Orphan designation status: Positive.
First published: 2020-02-13. Last updated: 2022-01-28.

Intended use: Treatment of AL amyloidosis

Source: https://www.ema.europa.eu/en/medicines/human/orphan-designations/eu-3-19-2222